EMA orphan designation EU/3/13/1166
Active substance: heterologous human adult liver-derived progenitor cells
Designated 2013-08-17. Orphan designation status: Positive.
First published: 2013-08-22. Last updated: 2024-10-15.

Intended use: Treatment of citrullinaemia type 2

Source: https://www.ema.europa.eu/en/medicines/human/orphan-designations/eu-3-13-1166